EMA orphan designation EU/3/25/3068
Active substance: 1-(3-Bromobenzyl)-N3-(3,4-dichlorophenyl)-1H-1,2,4-triazole-3,5-diamine
Designated 2025-06-20. Orphan designation status: Positive.
First published: 2025-07-25.

Intended use: Treatment of radiation induced maculopathy

Source: https://www.ema.europa.eu/en/medicines/human/orphan-designations/eu-3-25-3068